EMA orphan designation EU/3/18/1990
Active substance: dimethyl fumarate
Designated 2018-03-21. Orphan designation status: Positive.
First published: 2018-05-15. Last updated: 2021-07-08.

Intended use: Treatment of Friedreich’s ataxia

Source: https://www.ema.europa.eu/en/medicines/human/orphan-designations/eu-3-18-1990